EMA orphan designation EU/3/07/511
Active substance: 3-methoxy-pregnenolone
Designated 2007-12-04. Orphan designation status: Positive.
First published: 2008-07-01. Last updated: 2008-07-01.

Intended use: Treatment of spinal cord injury

Source: https://www.ema.europa.eu/en/medicines/human/orphan-designations/eu-3-07-511